EMA orphan designation EU/3/21/2531
Active substance: adeno-associated viral vector serotype 9 containing the human SURF1 gene
Designated 2021-11-12. Orphan designation status: Withdrawn.
First published: 2022-06-15.

Intended use: Treatment of Leigh syndrome

Source: https://www.ema.europa.eu/en/medicines/human/orphan-designations/eu-3-21-2531